EMA orphan designation EU/3/19/2147
Active substance: 2-[3-(2-chloro-4-{[5-cyclopropyl-3-(2,6-dichlorophenyl)-1,2-oxazol-4-yl]methoxy}phenyl)-3-hydroxyazetidin-1-yl]pyridine-4-carboxylic acid-2-amino-2-(hydroxymethyl)propane-1,3-diol (1/1)
Designated 2019-04-01. Orphan designation status: Withdrawn.
First published: 2019-05-07. Last updated: 2026-05-26.

Intended use: Treatment of primary sclerosing cholangitis

Source: https://www.ema.europa.eu/en/medicines/human/orphan-designations/eu-3-19-2147